EMA orphan designation EU/3/15/1549
Active substance: synthetic peptide L-cysteine, L-cysteinylglycyl-L-glutaminyl-L-arginyl-L-.alpha.-glutamyl-L-threonyl-L-prolyl-L-.alpha.-glutamylglycyl-L-alanyl-L-.alpha.-glutamyl-L-alanyl-L-lysyl-L-prolyl-L-tryptophyl-L-tyrosyl-, cyclic (1.fwdarw.17)-disulfide
Designated 2015-10-09. Orphan designation status: Positive.
First published: 2015-11-16. Last updated: 2015-11-16.

Intended use: Treatment of primary graft dysfunction following lung transplantation

Source: https://www.ema.europa.eu/en/medicines/human/orphan-designations/eu-3-15-1549